EMA orphan designation EU/3/25/3098
Active substance: human bispecific monoclonal antibody targeting ALK1 and BMPRII
Designated 2025-07-18. Orphan designation status: Positive.
First published: 2025-09-04.

Intended use: Treatment of hereditary haemorrhagic telangiectasia

Source: https://www.ema.europa.eu/en/medicines/human/orphan-designations/eu-3-25-3098